EMA orphan designation EU/3/14/1391
Active substance: 5-[8-methyl-9-(1-methylethyl)-2-(4-morpholinyl)-9H-purin-6-yl]-2-pyrimidinamine
Designated 2014-12-16. Orphan designation status: Withdrawn.
First published: 2015-02-26. Last updated: 2016-02-10.

Intended use: Treatment of malignant mesothelioma

Source: https://www.ema.europa.eu/en/medicines/human/orphan-designations/eu-3-14-1391